EMA orphan designation EU/3/09/671
Active substance: pasireotide
Designated 2009-10-08. Orphan designation status: Expired.
First published: 2009-10-20. Last updated: 2022-06-14.

Intended use: Treatment of Cushing's disease

Source: https://www.ema.europa.eu/en/medicines/human/orphan-designations/eu-3-09-671